EMA orphan designation EU/3/14/1426
Active substance: allogeneic peripheral blood mononuclear cells induced to an early apoptotic state
Designated 2015-01-15. Orphan designation status: Withdrawn.
First published: 2015-03-06. Last updated: 2022-02-28.

Intended use: Prevention of graft-versus-host disease

Source: https://www.ema.europa.eu/en/medicines/human/orphan-designations/eu-3-14-1426